EMA orphan designation EU/3/15/1557
Active substance: sirolimus
Designated 2015-10-09. Orphan designation status: Positive.
First published: 2015-11-18. Last updated: 2015-11-18.

Intended use: Treatment of tuberous sclerosis

Source: https://www.ema.europa.eu/en/medicines/human/orphan-designations/eu-3-15-1557